EMA orphan designation EU/3/24/2928
Active substance: sargramostim
Designated 2024-06-28. Orphan designation status: Positive.
First published: 2024-08-22.

Intended use: Treatment of pulmonary alveolar proteinosis

Source: https://www.ema.europa.eu/en/medicines/human/orphan-designations/eu-3-24-2928